EMA orphan designation EU/3/16/1785
Active substance: R-azasetron besilate
Designated 2016-11-18. Orphan designation status: Positive.
First published: 2016-12-13. Last updated: 2016-12-13.

Intended use: Treatment of sudden sensorineural hearing loss

Source: https://www.ema.europa.eu/en/medicines/human/orphan-designations/eu-3-16-1785